EMA orphan designation EU/3/17/1862
Active substance: autologous adult bone marrow-derived non-expanded CD133+ haematopoietic stem cells
Designated 2017-04-20. Orphan designation status: Positive.
First published: 2017-05-15. Last updated: 2020-03-31.

Intended use: Treatment of Asherman's syndrome

Source: https://www.ema.europa.eu/en/medicines/human/orphan-designations/eu-3-17-1862